EMA orphan designation EU/3/25/3103
Active substance: admilparant
Designated 2025-08-22. Orphan designation status: Positive.
First published: 2026-04-10.

Intended use: Treatment of idiopathic pulmonary fibrosis

Source: https://www.ema.europa.eu/en/medicines/human/orphan-designations/eu-3-25-3103